EMA orphan designation EU/3/22/2590
Active substance: alisporivir
Designated 2022-03-16. Orphan designation status: Positive.
First published: 2022-06-20. Last updated: 2022-12-14.

Intended use: Treatment of collagen VI-related myopathies

Source: https://www.ema.europa.eu/en/medicines/human/orphan-designations/eu-3-22-2590